EMA orphan designation EU/3/23/2757
Active substance: melatonin
Designated 2023-02-15. Orphan designation status: Positive.
First published: 2024-01-24.

Intended use: Treatment of perinatal asphyxia

Source: https://www.ema.europa.eu/en/medicines/human/orphan-designations/eu-3-23-2757